EMA orphan designation EU/3/15/1493
Active substance: allopurinol sodium
Designated 2015-05-21. Orphan designation status: Positive.
First published: 2015-06-30. Last updated: 2015-06-30.

Intended use: Treatment of perinatal asphyxia

Source: https://www.ema.europa.eu/en/medicines/human/orphan-designations/eu-3-15-1493